EMA orphan designation EU/3/05/347
Active substance: human monoclonal antibody against HLA-DR
Designated 2006-01-24. Orphan designation status: Withdrawn.
First published: 2008-07-29. Last updated: 2008-07-29.

Intended use: Treatment of chronic lymphocytic leukaemia

Source: https://www.ema.europa.eu/en/medicines/human/orphan-designations/eu-3-05-347